EMA orphan designation EU/3/12/993
Active substance: N-Hydroxy-4-(3-methyl-2-(S)-phenyl-butyrylamino) benzamide
Designated 2012-04-26. Orphan designation status: Positive.
First published: 2012-06-18. Last updated: 2022-07-05.

Intended use: Treatment of neurofibromatosis type 2

Source: https://www.ema.europa.eu/en/medicines/human/orphan-designations/eu-3-12-993